EMA orphan designation EU/3/16/1677
Active substance: diclofenamide
Designated 2016-06-27. Orphan designation status: Positive.
First published: 2016-07-26. Last updated: 2016-07-26.

Intended use: Treatment of periodic paralysis

Source: https://www.ema.europa.eu/en/medicines/human/orphan-designations/eu-3-16-1677